EMA orphan designation EU/3/22/2578
Active substance: aldesleukin
Designated 2022-02-24. Orphan designation status: Positive.
First published: 2022-04-29.

Intended use: Treatment of amyotrophic lateral sclerosis

Source: https://www.ema.europa.eu/en/medicines/human/orphan-designations/eu-3-22-2578